EMA orphan designation EU/3/22/2598
Active substance: adeno-associated virus vector serotype 9 encoding human gigaxonin gene
Designated 2022-04-13. Orphan designation status: Withdrawn.
First published: 2022-07-19. Last updated: 2025-09-04.

Intended use: Treatment of giant axonal neuropathy

Source: https://www.ema.europa.eu/en/medicines/human/orphan-designations/eu-3-22-2598